EMA orphan designation EU/3/22/2663
Active substance: sodium phenylbutyrate
Designated 2022-07-18. Orphan designation status: Positive.
First published: 2023-01-23.

Intended use: Treatment of maple syrup urine disease

Source: https://www.ema.europa.eu/en/medicines/human/orphan-designations/eu-3-22-2663